EMA orphan designation EU/3/24/2897
Active substance: repagermanium
Designated 2024-02-19. Orphan designation status: Positive.
First published: 2024-04-11.

Intended use: Treatment of focal segmental glomerulosclerosis

Source: https://www.ema.europa.eu/en/medicines/human/orphan-designations/eu-3-24-2897